EMA orphan designation EU/3/10/812
Active substance: recombinant fusion protein consisting of the extracellular portion of human activin receptor IIB linked to the human IgG1 Fc domain
Designated 2010-11-26. Orphan designation status: Withdrawn.
First published: 2010-12-17. Last updated: 2015-03-06.

Intended use: Treatment of Duchenne muscular dystrophy

Source: https://www.ema.europa.eu/en/medicines/human/orphan-designations/eu-3-10-812